EMA orphan designation EU/3/22/2575
Active substance: bovactant
Designated 2022-02-24. Orphan designation status: Positive.
First published: 2022-04-29.

Intended use: Treatment of respiratory distress syndrome

Source: https://www.ema.europa.eu/en/medicines/human/orphan-designations/eu-3-22-2575